EMA orphan designation EU/3/25/3147
Active substance: nangibotide
Designated 2025-11-21. Orphan designation status: Positive.
First published: 2026-04-14.

Intended use: Treatment of sickle cell disease

Source: https://www.ema.europa.eu/en/medicines/human/orphan-designations/eu-3-25-3147